EMA orphan designation EU/3/15/1589: Amglidia
Active substance: glibenclamide
Designated 2016-01-15. Orphan designation status: Positive. EMA product number: EMEA/H/C/004379.
First published: 2016-02-02. Last updated: 2019-01-04.

Intended use: Treatment of neonatal diabetes

Source: https://www.ema.europa.eu/en/medicines/human/orphan-designations/eu-3-15-1589